EMA orphan designation EU/3/11/917
Active substance: adeno-associated viral vector containing the human alpha-N-acetylglucosaminidase gene
Designated 2011-10-27. Orphan designation status: Positive.
First published: 2011-11-23. Last updated: 2011-11-23.

Intended use: Treatment of mucopolysaccharidosis type IIIB (Sanfilippo B syndrome)

Source: https://www.ema.europa.eu/en/medicines/human/orphan-designations/eu-3-11-917